EMA orphan designation EU/3/12/1090
Active substance: recombinant human monoclonal antibody of the IgG1 kappa class against prostate stem-cell antigen
Designated 2013-01-24. Orphan designation status: Positive.
First published: 2013-03-01. Last updated: 2014-10-16.

Intended use: Treatment of pancreatic cancer

Source: https://www.ema.europa.eu/en/medicines/human/orphan-designations/eu-3-12-1090